EMA orphan designation EU/3/22/2725
Active substance: Iodine (124I) evuzamitide
Designated 2022-12-09. Orphan designation status: Positive.
First published: 2023-03-13. Last updated: 2023-07-25.

Intended use: Diagnosis of ATTR amyloidosis

Source: https://www.ema.europa.eu/en/medicines/human/orphan-designations/eu-3-22-2725